EMA orphan designation EU/3/16/1729: Prohippur
Active substance: sodium benzoate
Designated 2016-08-29. Orphan designation status: Positive. EMA product number: EMEA/H/C/004150.
First published: 2016-10-03. Last updated: 2016-10-03.

Intended use: Treatment of lysinuric protein intolerance

Source: https://www.ema.europa.eu/en/medicines/human/orphan-designations/eu-3-16-1729